EMA orphan designation EU/3/12/976: Spinraza
Active substance: antisense oligonucleotide targeted to the SMN2 gene (nusinersen)
Designated 2012-04-02. Orphan designation status: Positive. EMA product number: EMEA/H/C/004312.
First published: 2012-04-30. Last updated: 2018-10-16.

Intended use: Treatment of 5q spinal muscular atrophy

Source: https://www.ema.europa.eu/en/medicines/human/orphan-designations/eu-3-12-976